EMA orphan designation EU/3/20/2370
Active substance: tremelimumab
Designated 2020-12-09. Orphan designation status: Withdrawn.
First published: 2021-05-21. Last updated: 2022-12-09.

Intended use: Treatment of hepatocellular carcinoma

Source: https://www.ema.europa.eu/en/medicines/human/orphan-designations/eu-3-20-2370